EMA orphan designation EU/3/06/387
Active substance: amikacin sulfate
Designated 2006-07-25. Orphan designation status: Positive.
First published: 2009-04-02. Last updated: 2020-11-03.

Intended use: Treatment of Pseudomonas aeruginosa lung infection in cystic fibrosis

Source: https://www.ema.europa.eu/en/medicines/human/orphan-designations/eu-3-06-387